EMA orphan designation EU/3/09/637
Active substance: 2',3',5'-Tri-O-acetyluridine
Designated 2009-05-15. Orphan designation status: Positive.
First published: 2009-06-09. Last updated: 2023-09-11.

Intended use: Treatment of 5-fluorouracil overdose

Source: https://www.ema.europa.eu/en/medicines/human/orphan-designations/eu-3-09-637